EMA orphan designation EU/3/10/811: Inrebic
Active substance: N-tert-butyl-3-[(5-methyl-2-{[4-(2-pyrrolidin-1-ylethoxy)phenyl]amino}pyrimidin-4-yl)amino] benzenesulfonamide dihydrochloride monohydrate (fedratinib)
Designated 2010-11-26. Orphan designation status: Positive. EMA product number: EMEA/H/C/005026.
First published: 2010-12-17. Last updated: 2021-09-17.

Intended use: Treatment of post-polycythaemia vera myelofibrosis

Source: https://www.ema.europa.eu/en/medicines/human/orphan-designations/eu-3-10-811